EMA orphan designation EU/3/13/1118: Brineura
Active substance: recombinant human tripeptidyl-peptidase 1
Designated 2013-03-12. Orphan designation status: Positive. EMA product number: EMEA/H/C/004065.
First published: 2013-04-05. Last updated: 2016-02-09.

Intended use: Treatment of neuronal ceroid lipofuscinosis type 2

Source: https://www.ema.europa.eu/en/medicines/human/orphan-designations/eu-3-13-1118